EMA orphan designation EU/3/06/410
Active substance: doxorubicin hydrochloride
Designated 2006-10-27. Orphan designation status: Positive.
First published: 2009-04-02. Last updated: 2009-04-02.

Intended use: Treatment of soft tissue sarcoma

Source: https://www.ema.europa.eu/en/medicines/human/orphan-designations/eu-3-06-410